EMA orphan designation EU/3/04/204
Active substance: aztreonam lysinate
Designated 2004-06-21. Orphan designation status: Expired.
First published: 2007-05-30. Last updated: 2019-10-16.

Intended use: Treatment of Gram-negative bacterial lung infection in cystic fibrosis

Source: https://www.ema.europa.eu/en/medicines/human/orphan-designations/eu-3-04-204